EMA orphan designation EU/3/10/754
Active substance: heparin-activated recombinant human fibroblast growth factor 1 (on a biodegradable device made from alpha-calcium sulfate hemihydrate)
Designated 2010-07-27. Orphan designation status: Withdrawn.
First published: 2010-08-05. Last updated: 2021-02-03.

Intended use: Treatment of traumatic spinal cord injury

Source: https://www.ema.europa.eu/en/medicines/human/orphan-designations/eu-3-10-754